EMA orphan designation EU/3/21/2438
Active substance: zanubrutinib
Designated 2021-05-20. Orphan designation status: Withdrawn.
First published: 2022-03-18. Last updated: 2023-01-05.

Intended use: Treatment of marginal zone lymphoma

Source: https://www.ema.europa.eu/en/medicines/human/orphan-designations/eu-3-21-2438